EMA orphan designation EU/3/12/1011: Xyndari
Active substance: Levoglutamide
Designated 2012-07-04. Orphan designation status: Positive. EMA product number: EMEA/H/C/004734.
First published: 2012-07-24. Last updated: 2019-07-12.

Intended use: Treatment of sickle cell disease

Source: https://www.ema.europa.eu/en/medicines/human/orphan-designations/eu-3-12-1011